EMA orphan designation EU/3/23/2811
Active substance: relacorilant
Designated 2023-07-25. Orphan designation status: Positive.
First published: 2024-02-01. Last updated: 2025-09-04.

Intended use: Treatment of ovarian cancer

Source: https://www.ema.europa.eu/en/medicines/human/orphan-designations/eu-3-23-2811